EMA orphan designation EU/3/10/741
Active substance: pralatrexate
Designated 2010-06-10. Orphan designation status: Positive.
First published: 2010-06-24. Last updated: 2011-03-10.

Intended use: Treatment of cutaneous T-cell lymphoma

Source: https://www.ema.europa.eu/en/medicines/human/orphan-designations/eu-3-10-741